EMA orphan designation EU/3/13/1222
Active substance: amatuximab
Designated 2014-01-16. Orphan designation status: Positive.
First published: 2014-03-21. Last updated: 2020-08-11.

Intended use: Treatment of malignant mesothelioma

Source: https://www.ema.europa.eu/en/medicines/human/orphan-designations/eu-3-13-1222